EMA orphan designation EU/3/16/1758
Active substance: P-ethoxy growth factor receptor-bound protein 2 antisense oligonucleotide
Designated 2016-10-14. Orphan designation status: Positive.
First published: 2016-11-15. Last updated: 2020-08-27.

Intended use: Treatment of acute myeloid leukaemia

Source: https://www.ema.europa.eu/en/medicines/human/orphan-designations/eu-3-16-1758